EMA orphan designation EU/3/23/2777
Active substance: autologous blood-derived tumour and hypoxia educated macrophages
Designated 2023-05-22. Orphan designation status: Positive.
First published: 2024-01-25.

Intended use: Treatment of spinal cord injury

Source: https://www.ema.europa.eu/en/medicines/human/orphan-designations/eu-3-23-2777